EMA orphan designation EU/3/16/1775
Active substance: alpha-tocopherol
Designated 2016-11-18. Orphan designation status: Positive.
First published: 2016-12-14. Last updated: 2016-12-14.

Intended use: treatment of facioscapulohumeral muscular dystrophy

Source: https://www.ema.europa.eu/en/medicines/human/orphan-designations/eu-3-16-1775